EMA orphan designation EU/3/22/2640: Attrogy
Active substance: diflunisal
Designated 2022-06-21. Orphan designation status: Withdrawn. EMA product number: EMEA/H/C/006248.
First published: 2022-09-22. Last updated: 2025-07-17.

Intended use: Treatment of ATTR amyloidosis

Source: https://www.ema.europa.eu/en/medicines/human/orphan-designations/eu-3-22-2640